EMA orphan designation EU/3/16/1722: Alsitek; Masitinib AB Science
Active substance: Masitinib mesilate
Designated 2016-08-29. Orphan designation status: Positive. EMA product number: EMEA/H/C/004398; EMEA/H/C/005897.
First published: 2016-10-03. Last updated: 2024-06-28.

Intended use: Treatment of amyotrophic lateral sclerosis

Source: https://www.ema.europa.eu/en/medicines/human/orphan-designations/eu-3-16-1722